EMA orphan designation EU/3/13/1231
Designated 2014-01-16. Orphan designation status: Positive.
First published: 2014-03-24. Last updated: 2024-04-11.

Intended use: Treatment of acute lymphoblastic leukaemia

Source: https://www.ema.europa.eu/en/medicines/human/orphan-designations/eu-3-13-1231